EMA orphan designation EU/3/19/2179
Active substance: sodium benzoate; sodium phenylacetate
Designated 2019-06-28. Orphan designation status: Positive.
First published: 2019-10-14.

Intended use: Treatment of hyperargininaemia

Source: https://www.ema.europa.eu/en/medicines/human/orphan-designations/eu-3-19-2179